EMA orphan designation EU/3/09/635
Active substance: treprostinil diethanolamine
Designated 2009-05-15. Orphan designation status: Positive.
First published: 2009-06-09. Last updated: 2023-02-13.

Intended use: Treatment of systemic sclerosis

Source: https://www.ema.europa.eu/en/medicines/human/orphan-designations/eu-3-09-635